EMA orphan designation EU/3/21/2534
Active substance: allogenic fetal mesenchymal stem cells
Designated 2021-12-10. Orphan designation status: Positive.
First published: 2022-05-05.

Intended use: Treatment of osteogenesis imperfecta

Source: https://www.ema.europa.eu/en/medicines/human/orphan-designations/eu-3-21-2534